EMA orphan designation EU/3/22/2665
Active substance: pyridoxal 5'-phosphate
Designated 2022-07-18. Orphan designation status: Positive.
First published: 2023-01-23.

Intended use: Treatment of pyridoxal 5'‐phosphate homeostasis protein deficiency

Source: https://www.ema.europa.eu/en/medicines/human/orphan-designations/eu-3-22-2665